EMA orphan designation EU/3/14/1400
Active substance: (1S,4R,5R,7S)-3,4-dibenzyl-2-oxo-6,8-dioxa-3-azabyciclo[3.2.1]octane-7-carboxylic acid-L-lysine
Designated 2014-12-16. Orphan designation status: Positive.
First published: 2015-02-26. Last updated: 2018-06-08.

Intended use: Treatment of neurotrophic keratitis

Source: https://www.ema.europa.eu/en/medicines/human/orphan-designations/eu-3-14-1400